EMA orphan designation EU/3/08/545
Designated 2008-05-08. Orphan designation status: Positive.
First published: 2009-09-17. Last updated: 2016-04-11.

Intended use: Treatment of congenital erythropoietic porphyria

Source: https://www.ema.europa.eu/en/medicines/human/orphan-designations/eu-3-08-545